EMA orphan designation EU/3/09/691
Active substance: human anthrax immunoglobulin
Designated 2009-11-18. Orphan designation status: Withdrawn.
First published: 2009-11-18. Last updated: 2016-08-11.

Intended use: Treatment of inhalation anthrax disease

Source: https://www.ema.europa.eu/en/medicines/human/orphan-designations/eu-3-09-691